EMA orphan designation EU/3/25/3080
Active substance: 2-(2-(2-[2-(4-Benzothiazol-2-yl-phenoxy)-ethoxy]-ethoxy)-ethoxy)-ethanol
Designated 2025-06-20. Orphan designation status: Positive.
First published: 2025-07-17.

Intended use: Treatment of amyotrophic lateral sclerosis

Source: https://www.ema.europa.eu/en/medicines/human/orphan-designations/eu-3-25-3080